EMA orphan designation EU/3/23/2859
Active substance: Adeno-associated viral vector serotype rh10 encoding miRNA against SOD1 mRNA
Designated 2023-11-08. Orphan designation status: Positive.
First published: 2024-02-01.

Intended use: Treatment of amyotrophic lateral sclerosis

Source: https://www.ema.europa.eu/en/medicines/human/orphan-designations/eu-3-23-2859